EMA orphan designation EU/3/20/2321
Active substance: adeno-associated viral vector serotype 3B encoding shortened human ATP7B
Designated 2020-08-21. Orphan designation status: Withdrawn.
First published: 2020-11-25.

Intended use: Treatment of Wilson's disease

Source: https://www.ema.europa.eu/en/medicines/human/orphan-designations/eu-3-20-2321